EMA orphan designation EU/3/16/1770: Nezglyal
Active substance: 5-[4-[2-(5-(1-hydroxyethyl)-2-pyridinyl)ethoxy]benzyl]-2,4-thiazolidinedione hydrochloride
Designated 2016-11-18. Orphan designation status: Positive. EMA product number: EMEA/H/C/005757.
First published: 2016-12-13. Last updated: 2024-01-26.

Intended use: Treatment of adrenoleukodystrophy

Source: https://www.ema.europa.eu/en/medicines/human/orphan-designations/eu-3-16-1770